EMA orphan designation EU/3/21/2560
Active substance: posnafusp alfa
Designated 2022-01-14. Orphan designation status: Positive.
First published: 2022-06-09. Last updated: 2025-06-05.

Intended use: Treatment of mucopolysaccharidosis type IIIA (Sanfilippo A syndrome)

Source: https://www.ema.europa.eu/en/medicines/human/orphan-designations/eu-3-21-2560